EMA orphan designation EU/3/11/851
Active substance: Glufosfamide
Designated 2011-04-15. Orphan designation status: Positive.
First published: 2011-04-26. Last updated: 2015-03-23.

Intended use: Treatment of pancreatic cancer

Source: https://www.ema.europa.eu/en/medicines/human/orphan-designations/eu-3-11-851